EMA orphan designation EU/3/12/1071
Active substance: canakinumab
Designated 2012-11-08. Orphan designation status: Withdrawn.
First published: 2012-12-17. Last updated: 2016-01-14.

Intended use: Treatment of tumour-necrosis-factor-receptor-associated periodic syndrome

Source: https://www.ema.europa.eu/en/medicines/human/orphan-designations/eu-3-12-1071